EMA orphan designation EU/3/20/2340
Active substance: 2-(2-(18F)fluoropyridin-4-yl)-9H-pyrrolo[2,3-b:4,5-c']dipyridine
Designated 2020-10-19. Orphan designation status: Positive.
First published: 2021-02-15.

Intended use: Diagnosis of corticobasal degeneration

Source: https://www.ema.europa.eu/en/medicines/human/orphan-designations/eu-3-20-2340